EMA orphan designation EU/3/14/1374
Active substance: (3S)-1-azabicyclo[2.2.2]oct-3-yl{2-[2-(4-fluorophenyl)-1,3-thiazol-4-yl]propan-2-yl}carbaNmate (venglustat)
Designated 2014-11-19. Orphan designation status: Positive.
First published: 2015-01-15. Last updated: 2023-07-18.

Intended use: Treatment of Gaucher disease

Source: https://www.ema.europa.eu/en/medicines/human/orphan-designations/eu-3-14-1374